EMA orphan designation EU/3/08/527
Active substance: recombinant human monoclonal antibody to human IL-1beta of the IgG1/K class
Designated 2008-02-05. Orphan designation status: Withdrawn.
First published: 2008-07-29. Last updated: 2015-02-12.

Intended use: Treatment of systemic-onset juvenile idiopathic arthritis

Source: https://www.ema.europa.eu/en/medicines/human/orphan-designations/eu-3-08-527